EMA orphan designation EU/3/15/1592
Active substance: -[4-Aminobutanoic acid-glycyl-L-glutaminyl-L-arginyl-L-.alpha.-glutamyl-L-threonyl-L-prolyl-L-.alpha.-glutamylglycyl-L-alanyl-L-.alpha.-glutamyl-L-alanyl-L-lysyl-L-prolyl-L-tryptophyl-L-tyrosyl-L-aspartyl](cyclo 1-Dgamma17)
Designated 2015-12-14. Orphan designation status: Positive.
First published: 2016-02-03. Last updated: 2016-02-03.

Intended use: Treatment of pseudohypoaldosteronism 1B

Source: https://www.ema.europa.eu/en/medicines/human/orphan-designations/eu-3-15-1592